EMA orphan designation EU/3/16/1726
Active substance: recombinant human acid alpha-glucosidase conjugated with mannose-6-phosphate analogues
Designated 2016-08-29. Orphan designation status: Positive.
First published: 2016-10-03. Last updated: 2016-10-03.

Intended use: Treatment of glycogen storage disease type II (Pompe's disease)

Source: https://www.ema.europa.eu/en/medicines/human/orphan-designations/eu-3-16-1726